EMA orphan designation EU/3/05/289
Active substance: pegylated arginine deiminase
Designated 2005-06-20. Orphan designation status: Positive.
First published: 2005-07-26. Last updated: 2005-07-26.

Intended use: Treatment of hepatocellular carcinoma

Source: https://www.ema.europa.eu/en/medicines/human/orphan-designations/eu-3-05-289